EMA orphan designation EU/3/23/2853
Active substance: riboflavin
Designated 2023-11-08. Orphan designation status: Positive.
First published: 2024-01-31.

Intended use: Treatment of medium-chain acyl-coenzyme A dehydrogenase deficiency

Source: https://www.ema.europa.eu/en/medicines/human/orphan-designations/eu-3-23-2853